EMA orphan designation EU/3/16/1800
Active substance: Dantrolene sodium
Designated 2016-12-12. Orphan designation status: Withdrawn.
First published: 2017-01-09. Last updated: 2026-05-26.

Intended use: Treatment of Wolfram syndrome

Source: https://www.ema.europa.eu/en/medicines/human/orphan-designations/eu-3-16-1800